EMA orphan designation EU/3/23/2798
Active substance: pemafibrate
Designated 2023-07-25. Orphan designation status: Positive.
First published: 2024-01-30.

Intended use: Treatment of primary biliary cholangitis

Source: https://www.ema.europa.eu/en/medicines/human/orphan-designations/eu-3-23-2798